EMA orphan designation EU/3/21/2535
Active substance: autologous T cells ex vivo modified with a lentiviral vector encoding a chimeric antigen receptor specific for CD1a
Designated 2021-12-10. Orphan designation status: Positive.
First published: 2022-04-13.

Intended use: Treatment of acute lymphoblastic leukaemia

Source: https://www.ema.europa.eu/en/medicines/human/orphan-designations/eu-3-21-2535